EMA orphan designation EU/3/12/1052
Active substance: Humanised monoclonal IgG4 antibody against tissue-factor-pathway inhibitor (concizumab)
Designated 2012-10-10. Orphan designation status: Withdrawn.
First published: 2012-11-16. Last updated: 2022-12-14.

Intended use: Treatment of haemophilia A

Source: https://www.ema.europa.eu/en/medicines/human/orphan-designations/eu-3-12-1052